EMA orphan designation EU/3/12/973: Mepsevii
Active substance: recombinant human beta-glucuronidase (vestronidase alfa)
Designated 2012-03-21. Orphan designation status: Positive. EMA product number: EMEA/H/C/004438.
First published: 2012-04-19. Last updated: 2020-11-11.

Intended use: Treatment of mucopolysaccharidosis type VII (Sly syndrome)

Source: https://www.ema.europa.eu/en/medicines/human/orphan-designations/eu-3-12-973